EMA orphan designation EU/3/19/2211
Active substance: besilesomab
Designated 2019-10-17. Orphan designation status: Positive.
First published: 2020-01-22. Last updated: 2022-12-14.

Intended use: Treatment in haematopoietic stem cell transplantation

Source: https://www.ema.europa.eu/en/medicines/human/orphan-designations/eu-3-19-2211